EMA orphan designation EU/3/24/2900
Active substance: autologous CD3-positive T-cells expressing a chimeric antigen receptor against B cell maturation agent
Designated 2024-02-19. Orphan designation status: Positive.
First published: 2024-04-11.

Intended use: Treatment of AL amyloidosis

Source: https://www.ema.europa.eu/en/medicines/human/orphan-designations/eu-3-24-2900